EMA orphan designation EU/3/04/223
Active substance: heparin sodium
Designated 2004-09-02. Orphan designation status: Withdrawn.
First published: 2005-07-01. Last updated: 2017-02-10.

Intended use: Treatment of idiopathic pulmonary fibrosis

Source: https://www.ema.europa.eu/en/medicines/human/orphan-designations/eu-3-04-223